EMA orphan designation EU/3/14/1415
Active substance: adenoviral vector serotype 5 containing the vascular endothelial growth factor D isoform (preprocessed short form) from a CMV promoter
Designated 2015-01-15. Orphan designation status: Positive.
First published: 2015-03-05. Last updated: 2015-03-05.

Intended use: Treatment of placental insufficiency

Source: https://www.ema.europa.eu/en/medicines/human/orphan-designations/eu-3-14-1415